EMA orphan designation EU/3/02/108
Active substance: myristolated-peptidyl-recombinant SCR1-3 of human complement receptor type I
Designated 2002-07-30. Orphan designation status: Withdrawn.
First published: 2009-09-23. Last updated: 2009-09-23.

Intended use: Prevention of post transplantation graft dysfunction

Source: https://www.ema.europa.eu/en/medicines/human/orphan-designations/eu-3-02-108